EMA orphan designation EU/3/09/676
Active substance: sequence-modified recombinant human factor VIIa
Designated 2009-10-08. Orphan designation status: Withdrawn.
First published: 2009-10-20. Last updated: 2016-01-19.

Intended use: Treatment of haemophilia B

Source: https://www.ema.europa.eu/en/medicines/human/orphan-designations/eu-3-09-676